EMA orphan designation EU/3/11/920
Active substance: Gallium (68Ga)-pasireotide tetraxetan
Designated 2011-10-27. Orphan designation status: Positive.
First published: 2011-11-23. Last updated: 2011-11-23.

Intended use: Diagnosis of gastro-entero-pancreatic neuroendocrine tumours

Source: https://www.ema.europa.eu/en/medicines/human/orphan-designations/eu-3-11-920